EMA orphan designation EU/3/18/1987
Active substance: vocimagene amiretrorepvec
Designated 2018-02-22. Orphan designation status: Positive.
First published: 2018-04-11. Last updated: 2024-10-11.

Intended use: Treatment of glioma

Source: https://www.ema.europa.eu/en/medicines/human/orphan-designations/eu-3-18-1987